EMA orphan designation EU/3/02/105
Active substance: carbamic acid (common)/[[4-[[3-[[4-[1-(4-hydroxyphenyl)-1-methyl-ethyl]phenoxy]methyl]phenyl]methoxy]-phenyl]iminomethyl]-,ethyl ester (amelubant)
Designated 2002-06-26. Orphan designation status: Withdrawn.
First published: 2005-11-29. Last updated: 2005-11-29.

Intended use: Treatment of cystic fibrosis

Source: https://www.ema.europa.eu/en/medicines/human/orphan-designations/eu-3-02-105